EMA orphan designation EU/3/03/154
Active substance: Hydroxyurea
Designated 2003-07-09. Orphan designation status: Expired.
First published: 2008-07-29. Last updated: 2017-08-06.

Intended use: Treatment of sickle cell syndrome

Source: https://www.ema.europa.eu/en/medicines/human/orphan-designations/eu-3-03-154